EMA orphan designation EU/3/21/2475
Active substance: Infigratinib
Designated 2021-07-19. Orphan designation status: Positive.
First published: 2021-11-16.

Intended use: Treatment of achondroplasia

Source: https://www.ema.europa.eu/en/medicines/human/orphan-designations/eu-3-21-2475